EMA orphan designation EU/3/12/954
Active substance: S[+] apomorphine
Designated 2012-02-09. Orphan designation status: Positive.
First published: 2012-03-01. Last updated: 2019-10-03.

Intended use: Treatment of amyotrophic lateral sclerosis

Source: https://www.ema.europa.eu/en/medicines/human/orphan-designations/eu-3-12-954